EMA orphan designation EU/3/09/652
Active substance: ciprofloxacin
Designated 2009-07-24. Orphan designation status: Positive.
First published: 2009-09-11. Last updated: 2022-04-06.

Intended use: Treatment of cystic fibrosis

Source: https://www.ema.europa.eu/en/medicines/human/orphan-designations/eu-3-09-652